EMA orphan designation EU/3/18/2104
Active substance: (4-{(2S,4S)-4-ethoxy-1-[(5-methoxy-7-methyl-1H-indol-4-yl)methyl]piperidin-2-yl}benzoic acid-hydrogen chloride(1/1))
Designated 2018-12-14. Orphan designation status: Positive.
First published: 2019-03-11.

Intended use: Treatment of C3 glomerulopathy

Source: https://www.ema.europa.eu/en/medicines/human/orphan-designations/eu-3-18-2104